EMA orphan designation EU/3/23/2751
Active substance: autologous CD34+ cells transduced with a lentiviral vector containing the human GAA gene
Orphan designation status: Positive.
First published: 2024-01-19.

Intended use: Treatment of glycogen storage disease type II (Pompe's disease)

Source: https://www.ema.europa.eu/en/medicines/human/orphan-designations/eu-3-23-2751